EMA orphan designation EU/3/20/2324
Active substance: 3-(((1S,2S,3R)-2,3-difluoro-1-hydroxy-7-(methylsulfonyl)-2,3-dihydro-1H-inden-4-yl)oxy)-5-fluorobenzonitrile
Designated 2020-08-21. Orphan designation status: Withdrawn.
First published: 2020-11-25. Last updated: 2024-04-09.

Intended use: Treatment of von Hippel-Lindau disease

Source: https://www.ema.europa.eu/en/medicines/human/orphan-designations/eu-3-20-2324